EMA orphan designation EU/3/16/1706: Prohippur
Active substance: sodium benzoate
Designated 2016-07-14. Orphan designation status: Positive. EMA product number: EMEA/H/C/004150.
First published: 2016-09-06. Last updated: 2016-09-06.

Intended use: Treatment of carbamoyl-phosphate synthase-1 deficiency

Source: https://www.ema.europa.eu/en/medicines/human/orphan-designations/eu-3-16-1706